EMA orphan designation EU/3/24/3023
Active substance: N-(2-Methoxyethyl)-6-methyl-N-[(3-methyl-2-thienyl)methyl]-2-oxo-1,2-dihydropyridine-4-carboxamide
Designated 2025-01-16. Orphan designation status: Positive.
First published: 2025-02-20.

Intended use: Treatment of glycogen storage disease type IV

Source: https://www.ema.europa.eu/en/medicines/human/orphan-designations/eu-3-24-3023